EMA orphan designation EU/3/07/444: Folotyn
Active substance: pralatrexate
Designated 2007-04-13. Orphan designation status: Positive. EMA product number: EMEA/H/C/002096.
First published: 2008-07-02. Last updated: 2011-04-07.

Intended use: Treatment of peripheral T-cell lymphoma (nodal, other extranodal and leukaemic/disseminated)

Source: https://www.ema.europa.eu/en/medicines/human/orphan-designations/eu-3-07-444